EMA orphan designation EU/3/01/028
Active substance: Inolimomab
Designated 2001-03-05. Orphan designation status: Positive.
First published: 2008-08-18. Last updated: 2017-10-26.

Intended use: Treatment of graft-versus-host disease

Source: https://www.ema.europa.eu/en/medicines/human/orphan-designations/eu-3-01-028